EMA orphan designation EU/3/14/1307
Active substance: retinol
Designated 2014-08-22. Orphan designation status: Positive.
First published: 2014-09-29. Last updated: 2014-09-29.

Intended use: Prevention of bronchopulmonary dysplasia

Source: https://www.ema.europa.eu/en/medicines/human/orphan-designations/eu-3-14-1307